EMA orphan designation EU/3/07/524
Active substance: (R)-2-Methyl-6-nitro-2-{4-[4-(4-trifluoromethoxyphenoxy)piperidin-1-yl]phenoxymethyl}-2,3-dihydroimidazo[2,1-b]oxazole (delamanid)
Designated 2008-02-02. Orphan designation status: Expired.
First published: 2008-07-10. Last updated: 2014-06-18.

Intended use: Treatment of tuberculosis

Source: https://www.ema.europa.eu/en/medicines/human/orphan-designations/eu-3-07-524